EMA orphan designation EU/3/17/1831
Active substance: 505 amino acid protein, corresponding to amino acids 2-506 of the wild type human histidyl-tRNA synthetase
Designated 2017-02-27. Orphan designation status: Withdrawn.
First published: 2017-03-13. Last updated: 2020-02-12.

Intended use: Treatment of limb-girdle muscular dystrophy

Source: https://www.ema.europa.eu/en/medicines/human/orphan-designations/eu-3-17-1831